EMA orphan designation EU/3/19/2181
Active substance: 7-ethyl-10-hydroxy-camptothecin
Designated 2019-07-25. Orphan designation status: Positive.
First published: 2019-09-24. Last updated: 2023-06-09.

Intended use: Treatment of soft tissue sarcoma

Source: https://www.ema.europa.eu/en/medicines/human/orphan-designations/eu-3-19-2181